EMA orphan designation EU/3/22/2645
Active substance: Fosmanogepix
Designated 2022-07-18. Orphan designation status: Positive.
First published: 2023-01-23. Last updated: 2024-10-02.

Intended use: Treatment of invasive aspergillosis

Source: https://www.ema.europa.eu/en/medicines/human/orphan-designations/eu-3-22-2645